EMA orphan designation EU/3/15/1489
Active substance: Fusion proteins composed by a genetically modified cholera toxin subunit A1, peptides from the acetylcholine receptor alpha chain and a dimer of the D fragment from Staphylococcus aureus protein A
Designated 2015-05-21. Orphan designation status: Withdrawn.
First published: 2015-06-30. Last updated: 2019-04-12.

Intended use: Treatment of myasthenia gravis

Source: https://www.ema.europa.eu/en/medicines/human/orphan-designations/eu-3-15-1489